EMA orphan designation EU/3/13/1186
Active substance: Idelalisib
Designated 2013-08-05. Orphan designation status: Withdrawn.
First published: 2013-09-16. Last updated: 2013-11-18.

Intended use: Treatment of extranodal marginal-zone lymphoma of mucosa-associated lymphoid tissue (MALT lymphoma)

Source: https://www.ema.europa.eu/en/medicines/human/orphan-designations/eu-3-13-1186